EMA orphan designation EU/3/14/1372: Vynpenta (previously AvacopanChemoCentryx); Tavneos
Active substance: (2R,3S)-2-(4-cyclopentylaminophenyl)-1-(2-fluoro-6-methylbenzoyl)piperidine-3-carboxylic acid(4-methyl-3-trifluoromethylphenyl)amNide
Designated 2014-11-19. Orphan designation status: Positive. EMA product number: EMEA/H/C/004487; EMEA/H/C/005523.
First published: 2015-01-15. Last updated: 2022-07-04.

Intended use: Treatment of microscopic polyangiitis (avacopan)

Source: https://www.ema.europa.eu/en/medicines/human/orphan-designations/eu-3-14-1372